EMA orphan designation EU/3/26/3223
Active substance: Momelotinib dihydrochloride monohydrate
Designated 2026-04-20. Orphan designation status: Positive.
First published: 2026-04-22.

Intended use: Treatment of VEXAS syndrome

Source: https://www.ema.europa.eu/en/medicines/human/orphan-designations/eu-3-26-3223